EMA orphan designation EU/3/24/3025
Active substance: [4-(Methyl-1H-pyrazol-4-yl)-benzyl]-(6[7-(3-pyrrolidin-1-yl-propoxy)-imidazo[1,2-a]pyridin-3-yl]-pyrimidin-4-yl]-amine
Designated 2025-01-16. Orphan designation status: Positive.
First published: 2025-02-20.

Intended use: Treatment of gastrointestinal stromal tumours

Source: https://www.ema.europa.eu/en/medicines/human/orphan-designations/eu-3-24-3025